EMA orphan designation EU/3/12/1084
Active substance: Erdosteine
Designated 2012-12-06. Orphan designation status: Positive.
First published: 2013-01-25. Last updated: 2013-01-25.

Intended use: Treatment of lead toxicity

Source: https://www.ema.europa.eu/en/medicines/human/orphan-designations/eu-3-12-1084